EMA orphan designation EU/3/22/2620
Active substance: pasireotide
Designated 2022-05-16. Orphan designation status: Positive.
First published: 2022-12-14.

Intended use: Treatment of noninsulinoma pancreatogenous hypoglycemia syndrome

Source: https://www.ema.europa.eu/en/medicines/human/orphan-designations/eu-3-22-2620